EMA orphan designation EU/3/18/2087
Active substance: Etamsylate
Designated 2018-11-19. Orphan designation status: Positive.
First published: 2019-02-25. Last updated: 2020-06-08.

Intended use: Treatment of hereditary haemorrhagic telangiectasia

Source: https://www.ema.europa.eu/en/medicines/human/orphan-designations/eu-3-18-2087